EMA orphan designation EU/3/16/1807
Active substance: udenafil
Designated 2016-12-12. Orphan designation status: Positive.
First published: 2017-01-10. Last updated: 2017-01-10.

Intended use: Treatment of functional single ventricle congenital heart disease

Source: https://www.ema.europa.eu/en/medicines/human/orphan-designations/eu-3-16-1807